EMA orphan designation EU/3/10/765: Rydapt
Active substance: Midostaurin
Designated 2010-08-04. Orphan designation status: Positive. EMA product number: EMEA/H/C/004095.
First published: 2010-08-11. Last updated: 2018-05-29.

Intended use: Treatment of mastocytosis

Source: https://www.ema.europa.eu/en/medicines/human/orphan-designations/eu-3-10-765